EMA orphan designation EU/3/26/3228
Active substance: coagulation factor V
Designated 2026-04-20. Orphan designation status: Positive.
First published: 2026-04-22.

Intended use: Treatment of congenital factor V deficiency

Source: https://www.ema.europa.eu/en/medicines/human/orphan-designations/eu-3-26-3228